EMA orphan designation EU/3/00/006
Active substance: 1,5-(Butylimino)-1,5-dideoxy, D-glucitol
Designated 2000-10-18. Orphan designation status: Expired.
First published: 2009-05-15. Last updated: 2013-02-27.

Intended use: Treatment of Gaucher disease

Source: https://www.ema.europa.eu/en/medicines/human/orphan-designations/eu-3-00-006